EMA orphan designation EU/3/18/1973
Active substance: ultevursen
Designated 2018-02-22. Orphan designation status: Positive.
First published: 2018-04-10. Last updated: 2024-10-02.

Intended use: Treatment of retinitis pigmentosa

Source: https://www.ema.europa.eu/en/medicines/human/orphan-designations/eu-3-18-1973